EMA orphan designation EU/3/12/982
Active substance: Dipalmitoylphosphatidylcholine, 1-palmitoyl-2-oleoyl-sn-glycero-3-phosphoglycerol, sodium salt, synthetic surfactant protein C analogue and synthetic surfactant protein B analogue
Designated 2012-04-02. Orphan designation status: Positive.
First published: 2012-04-30. Last updated: 2012-04-30.

Intended use: Respiratory distress syndrome in premature neonates of less than 37 weeks of gestational age

Source: https://www.ema.europa.eu/en/medicines/human/orphan-designations/eu-3-12-982